EMA orphan designation EU/3/13/1150
Active substance: moxetumomab pasudotox
Orphan designation status: Withdrawn.
First published: 2013-08-22. Last updated: 2022-07-25.

Intended use: Treatment of B-lymphoblastic leukaemia/lymphoma

Source: https://www.ema.europa.eu/en/medicines/human/orphan-designations/eu-3-13-1150